EMA orphan designation EU/3/01/080
Active substance: human engineered monoclonal antibody specific for transforming growth factor ß1
Designated 2002-02-04. Orphan designation status: Withdrawn.
First published: 2007-02-23. Last updated: 2007-02-23.

Intended use: Treatment of systemic sclerosis

Source: https://www.ema.europa.eu/en/medicines/human/orphan-designations/eu-3-01-080